EMA orphan designation EU/3/24/2899
Active substance: Plerixafor
Designated 2024-02-19. Orphan designation status: Positive.
First published: 2024-04-11.

Intended use: Treatment of acute respiratory distress syndrome (ARDS)

Source: https://www.ema.europa.eu/en/medicines/human/orphan-designations/eu-3-24-2899